EMA orphan designation EU/3/06/392
Active substance: human monoclonal antibody against inhibitory killer cell lg-like receptors (1-7 F9)
Designated 2006-08-28. Orphan designation status: Withdrawn.
First published: 2009-04-24. Last updated: 2014-01-13.

Intended use: Treatment of acute myeloid leukaemia

Source: https://www.ema.europa.eu/en/medicines/human/orphan-designations/eu-3-06-392